EMA orphan designation EU/3/09/672
Active substance: Pomalidomide
Designated 2009-10-08. Orphan designation status: Expired.
First published: 2009-10-20. Last updated: 2023-09-13.

Intended use: Treatment of multiple myeloma

Source: https://www.ema.europa.eu/en/medicines/human/orphan-designations/eu-3-09-672